EMA orphan designation EU/3/22/2701
Active substance: isotretinoin
Designated 2022-10-11. Orphan designation status: Withdrawn.
First published: 2023-01-24. Last updated: 2025-01-15.

Intended use: Treatment of autosomal recessive congenital ichthyosis

Source: https://www.ema.europa.eu/en/medicines/human/orphan-designations/eu-3-22-2701